EMA orphan designation EU/3/09/722
Active substance: pixantrone dimaleate
Designated 2010-02-02. Orphan designation status: Withdrawn.
First published: 2010-03-04. Last updated: 2011-05-18.

Intended use: Treatment of diffuse large B-cell lymphoma

Source: https://www.ema.europa.eu/en/medicines/human/orphan-designations/eu-3-09-722